EMA orphan designation EU/3/09/670
Active substance: pasireotide
Designated 2009-10-08. Orphan designation status: Expired.
First published: 2009-10-20. Last updated: 2024-11-28.

Intended use: Treatment of acromegaly

Source: https://www.ema.europa.eu/en/medicines/human/orphan-designations/eu-3-09-670